EMA orphan designation EU/3/09/680
Active substance: 5'-O-(trans-9''-octadecenoyl)-1-beta-D-2'-deoxy-2',2'-difluorocytidine
Designated 2009-10-28. Orphan designation status: Withdrawn.
First published: 2009-11-18. Last updated: 2013-06-24.

Intended use: Treatment of pancreatic cancer

Source: https://www.ema.europa.eu/en/medicines/human/orphan-designations/eu-3-09-680